EMA orphan designation EU/3/24/2952
Active substance: N-[(1R)-1-[(S)-(2-Chloro-3-fluorophenyl)hydroxymethyl]butyl]-7-fluoro-2,3-dihydro-2-oxo-1H-indole-4-carboxamide
Designated 2024-07-25. Orphan designation status: Positive.
First published: 2024-09-13.

Intended use: Treatment of congenital alpha-1 antitrypsin deficiency

Source: https://www.ema.europa.eu/en/medicines/human/orphan-designations/eu-3-24-2952